EMA orphan designation EU/3/02/097
Active substance: Humanized anti-KSA monoclonal antibody - human interleukin-2-fusion protein
Designated 2002-03-22. Orphan designation status: Withdrawn.
First published: 2003-05-23. Last updated: 2003-05-23.

Intended use: Treatment of renal-cell carcinoma

Source: https://www.ema.europa.eu/en/medicines/human/orphan-designations/eu-3-02-097